EMA orphan designation EU/3/16/1725
Active substance: recombinant protein derived from the saliva of the Ornithodoros moubata tick (nomacopan)
Designated 2016-08-29. Orphan designation status: Positive.
First published: 2016-10-03. Last updated: 2022-01-26.

Intended use: Treatment of paroxysmal nocturnal haemoglobinuria

Source: https://www.ema.europa.eu/en/medicines/human/orphan-designations/eu-3-16-1725